EMA orphan designation EU/3/17/1905
Active substance: purified pasteurised and freeze-dried cell-wall fragments from Mycobacterium tuberculosis strain RUTI
Designated 2017-08-23. Orphan designation status: Positive.
First published: 2017-10-17. Last updated: 2017-10-17.

Intended use: Treatment of tuberculosis

Source: https://www.ema.europa.eu/en/medicines/human/orphan-designations/eu-3-17-1905